EMA orphan designation EU/3/13/1140
Active substance: expanded human allogeneic neural retinal progenitor cells extracted from neural retina
Designated 2013-06-19. Orphan designation status: Positive.
First published: 2013-07-11. Last updated: 2013-07-11.

Intended use: Treatment of retinitis pigmentosa

Source: https://www.ema.europa.eu/en/medicines/human/orphan-designations/eu-3-13-1140